EMA orphan designation EU/3/13/1215
Active substance: (4R,5R)-1-[[4-[[4-[3,3-dibutyl-7-(dimethylamino)-2,3,4,5- tetrahydro-4-hydroxy-1,1-dioxido-1-benzothiepin-5-yl]phenoxy]methyl]phenyl]methyl]-4-aza-1-azoniabicyclo[2.2.2]octane chloride (maralixibat chloride)
Designated 2014-01-16. Orphan designation status: Withdrawn.
First published: 2014-01-22. Last updated: 2022-06-14.

Intended use: Treatment of primary biliary cirrhosis

Source: https://www.ema.europa.eu/en/medicines/human/orphan-designations/eu-3-13-1215